EMA orphan designation EU/3/10/810: Inrebic
Active substance: N-tert-butyl-3-[(5-methyl-2-{[4-(2-pyrrolidin-1-ylethoxy)phenyl]amino}pyrimidin-4-yl)amino] benzenesulfonamide dihydrochloride monohydrate (fedratinib)
Designated 2010-11-26. Orphan designation status: Positive. EMA product number: EMEA/H/C/005026.
First published: 2010-12-17. Last updated: 2021-09-17.

Intended use: Treatment of post-essential thrombocythaemia myelofibrosis

Source: https://www.ema.europa.eu/en/medicines/human/orphan-designations/eu-3-10-810